EMA orphan designation EU/3/06/399
Active substance: mecasermin rinfabate
Designated 2006-08-28. Orphan designation status: Positive.
First published: 2009-04-24. Last updated: 2024-10-15.

Intended use: Prevention of retinopathy of prematurity in neonates of less than 32 weeks of gestational age

Source: https://www.ema.europa.eu/en/medicines/human/orphan-designations/eu-3-06-399